EMA orphan designation EU/3/05/298
Active substance: mifepristone
Designated 2005-07-27. Orphan designation status: Withdrawn.
First published: 2005-10-11. Last updated: 2017-02-10.

Intended use: Treatment of Cushing's syndrome secondary to ectopic ACTH secretion

Source: https://www.ema.europa.eu/en/medicines/human/orphan-designations/eu-3-05-298